EMA orphan designation EU/3/01/029
Active substance: ribavirin
Designated 2001-03-08. Orphan designation status: Withdrawn.
First published: 2009-03-03. Last updated: 2011-05-07.

Intended use: Treatment of adenovirus infection in immunocompromised patients

Source: https://www.ema.europa.eu/en/medicines/human/orphan-designations/eu-3-01-029